EMA orphan designation EU/3/18/2082
Active substance: 5-{(1R,2R)-2-[(cyclopropylmethyl)amino]cyclopropyl}-N-(tetrahydro-2H-pyran-4-yl)thiophene-3-carboxamide monohydrochloride
Designated 2018-11-19. Orphan designation status: Withdrawn.
First published: 2019-02-19. Last updated: 2022-11-22.

Intended use: Treatment of Kabuki syndrome

Source: https://www.ema.europa.eu/en/medicines/human/orphan-designations/eu-3-18-2082